EMA orphan designation EU/3/14/1291
Active substance: rilotumumab
Designated 2014-07-29. Orphan designation status: Withdrawn.
First published: 2014-09-01. Last updated: 2014-09-01.

Intended use: Treatment of gastric cancer

Source: https://www.ema.europa.eu/en/medicines/human/orphan-designations/eu-3-14-1291